EMA orphan designation EU/3/23/2765
Active substance: anagrelide hydrochloride monohydrate
Designated 2023-03-20. Orphan designation status: Positive.
First published: 2024-02-01.

Intended use: Treatment of gastrointestinal stromal tumours

Source: https://www.ema.europa.eu/en/medicines/human/orphan-designations/eu-3-23-2765